EMA orphan designation EU/3/23/2833
Active substance: setmelanotide
Designated 2023-10-13. Orphan designation status: Positive.
First published: 2024-02-01.

Intended use: Treatment of acquired hypothalamic obesity

Source: https://www.ema.europa.eu/en/medicines/human/orphan-designations/eu-3-23-2833